EMA orphan designation EU/3/21/2424
Active substance: cevostamab
Designated 2021-04-13. Orphan designation status: Positive.
First published: 2022-01-24.

Intended use: Treatment of multiple myeloma

Source: https://www.ema.europa.eu/en/medicines/human/orphan-designations/eu-3-21-2424